EMA orphan designation EU/3/12/967
Active substance: sodium nitrite
Designated 2012-03-05. Orphan designation status: Positive.
First published: 2012-07-06. Last updated: 2012-07-06.

Intended use: Treatment of pulmonary arterial hypertension

Source: https://www.ema.europa.eu/en/medicines/human/orphan-designations/eu-3-12-967